EMA orphan designation EU/3/01/064
Active substance: abetimus sodium
Designated 2001-11-20. Orphan designation status: Withdrawn.
First published: 2009-03-03. Last updated: 2011-05-31.

Intended use: Treatment of lupus nephritis

Source: https://www.ema.europa.eu/en/medicines/human/orphan-designations/eu-3-01-064